EMA orphan designation EU/3/25/3174
Active substance: amsulostat
Designated 2025-12-09. Orphan designation status: Positive.
First published: 2026-03-27.

Intended use: Treatment of myelofibrosis

Source: https://www.ema.europa.eu/en/medicines/human/orphan-designations/eu-3-25-3174